EMA orphan designation EU/3/17/1875
Active substance: recombinant human interleukin-7 fused to a hybrid crystallisable fragment region of a human antibody-
Designated 2017-05-22. Orphan designation status: Positive.
First published: 2017-06-29. Last updated: 2017-06-29.

Intended use: Treatment of idiopathic CD4 lymphocytopenia

Source: https://www.ema.europa.eu/en/medicines/human/orphan-designations/eu-3-17-1875